EMA orphan designation EU/3/13/1128
Active substance: N-[2,6-bis(1-methylethyl)phenyl]-N'-[[1-[4-(dimethylamino) phenyl]cyclopentyl]methyl]urea, hydrochloride salt
Designated 2013-06-07. Orphan designation status: Positive.
First published: 2013-06-25. Last updated: 2017-11-13.

Intended use: Treatment of adrenocortical carcinoma

Source: https://www.ema.europa.eu/en/medicines/human/orphan-designations/eu-3-13-1128